EMA orphan designation EU/3/25/3075
Active substance: Exidavnemab
Designated 2025-06-20. Orphan designation status: Positive.
First published: 2025-07-25.

Intended use: Treatment of multiple system atrophy

Source: https://www.ema.europa.eu/en/medicines/human/orphan-designations/eu-3-25-3075